EMA orphan designation EU/3/01/052
Active substance: seocalcitol
Designated 2001-07-31. Orphan designation status: Withdrawn.
First published: 2004-11-08. Last updated: 2004-11-08.

Intended use: Treatment of hepatocellular carcinoma

Source: https://www.ema.europa.eu/en/medicines/human/orphan-designations/eu-3-01-052